EMA orphan designation EU/3/19/2217
Active substance: propranolol hydrochloride
Designated 2019-10-17. Orphan designation status: Positive.
First published: 2020-01-23.

Intended use: Treatment of retinopathy of prematurity

Source: https://www.ema.europa.eu/en/medicines/human/orphan-designations/eu-3-19-2217